EMA orphan designation EU/3/17/1840
Active substance: N-(4-(1-cyanocyclopentyl)phenyl)-2-(4-pyridinylmethyl)amino-3-pyridinecarboxamide methanesulfonate
Designated 2017-02-27. Orphan designation status: Positive.
First published: 2017-03-31. Last updated: 2021-03-02.

Intended use: Treatment of gastric cancer

Source: https://www.ema.europa.eu/en/medicines/human/orphan-designations/eu-3-17-1840